EMA orphan designation EU/3/13/1183
Active substance: sacrosidase
Designated 2013-08-05. Orphan designation status: Positive.
First published: 2013-09-16. Last updated: 2014-04-03.

Intended use: Treatment of congenital sucrase-isomaltase deficiency

Source: https://www.ema.europa.eu/en/medicines/human/orphan-designations/eu-3-13-1183